EMA orphan designation EU/3/16/1762
Active substance: synthetic 15-amino-acid macrocyclic peptide acylated with a polyethyleneglycol palmitoylated linker (zilucoplan)
Designated 2016-10-14. Orphan designation status: Withdrawn.
First published: 2016-11-15. Last updated: 2022-02-01.

Intended use: Treatment of paroxysmal nocturnal haemoglobinuria

Source: https://www.ema.europa.eu/en/medicines/human/orphan-designations/eu-3-16-1762